EMA orphan designation EU/3/12/1086: Flynpovi
Active substance: eflornithine
Designated 2013-01-24. Orphan designation status: Positive. EMA product number: EMEA/H/C/005043.
First published: 2013-03-01. Last updated: 2019-04-29.

Intended use: Treatment of familial adenomatous polyposis (FAP)

Source: https://www.ema.europa.eu/en/medicines/human/orphan-designations/eu-3-12-1086